EMA orphan designation EU/3/19/2182: Iqirvo
Active substance: Elafibranor
Designated 2019-07-25. Orphan designation status: Positive. EMA product number: EMEA/H/C/006231.
First published: 2019-09-25. Last updated: 2022-09-08.

Intended use: Treatment of primary biliary cholangitis

Source: https://www.ema.europa.eu/en/medicines/human/orphan-designations/eu-3-19-2182